EMA orphan designation EU/3/18/2073
Active substance: autologous CD34+ haematopoietic stem and progenitor cells genetically modified with the lentiviral vector IDUA LV, encoding for the alpha-L-iduronidase cDNA
Designated 2018-10-26. Orphan designation status: Positive.
First published: 2019-01-10. Last updated: 2022-07-14.

Intended use: Treatment of mucopolysaccharidosis type I

Source: https://www.ema.europa.eu/en/medicines/human/orphan-designations/eu-3-18-2073